EMA orphan designation EU/3/12/1000
Active substance: polyinosine-polycytidylic acid coupled with the polycationic polyethyleneimine
Designated 2012-06-06. Orphan designation status: Withdrawn.
First published: 2012-07-04. Last updated: 2013-09-23.

Intended use: Treatment of pancreatic cancer

Source: https://www.ema.europa.eu/en/medicines/human/orphan-designations/eu-3-12-1000